EMA orphan designation EU/3/09/669
Active substance: dextran sulfate
Designated 2009-10-09. Orphan designation status: Positive.
First published: 2009-10-20. Last updated: 2011-11-18.

Intended use: Prevention of graft rejection during pancreatic islet transplantation

Source: https://www.ema.europa.eu/en/medicines/human/orphan-designations/eu-3-09-669